EMA orphan designation EU/3/20/2367
Active substance: (R)-3-(1-(2,3-dichloro-4-(pyrazin-2-yl)phenyl)-2,2,2-trifluoroethyl)-1-methyl-1-(1-methylpiperidin-4-yl)urea fumarate
Designated 2020-12-09. Orphan designation status: Positive.
First published: 2021-05-19.

Intended use: Treatment of Prader-Willi syndrome

Source: https://www.ema.europa.eu/en/medicines/human/orphan-designations/eu-3-20-2367